EMA orphan designation EU/3/14/1356: Luxceptar
Active substance: Donor T lymphocytes depleted ex-vivo of host alloreactive T cells using photodynamic treatment
Designated 2014-11-19. Orphan designation status: Withdrawn. EMA product number: EMEA/H/C/002397.
First published: 2015-01-15. Last updated: 2022-02-28.

Intended use: Treatment of acute myeloid leukaemia

Source: https://www.ema.europa.eu/en/medicines/human/orphan-designations/eu-3-14-1356